EMA orphan designation EU/3/10/740
Active substance: perifosine
Designated 2010-06-10. Orphan designation status: Withdrawn.
First published: 2010-06-24. Last updated: 2014-02-07.

Intended use: Treatment of multiple myeloma

Source: https://www.ema.europa.eu/en/medicines/human/orphan-designations/eu-3-10-740